EMA orphan designation EU/3/23/2849
Active substance: ambroxol hydrochloride
Designated 2023-11-08. Orphan designation status: Positive.
First published: 2024-02-01.

Intended use: Treatment of Gaucher disease

Source: https://www.ema.europa.eu/en/medicines/human/orphan-designations/eu-3-23-2849